EMA orphan designation EU/3/10/786
Active substance: Heat-killed Mycobacterium vaccae (whole cell)
Designated 2010-09-20. Orphan designation status: Positive.
First published: 2010-10-18. Last updated: 2022-03-08.

Intended use: Treatment of tuberculosis

Source: https://www.ema.europa.eu/en/medicines/human/orphan-designations/eu-3-10-786